EMA orphan designation EU/3/20/2281: Fabhalta
Active substance: (4-{(2S,4S)-4-ethoxy-1-[(5-methoxy-7-methyl-1H-indol-4-yl)methyl]piperidin-2-yl}benzoic acid-hydrogen chloride(1/1))
Designated 2020-06-04. Orphan designation status: Positive. EMA product number: EMEA/H/C/005764.
First published: 2020-08-19.

Intended use: Treatment of paroxysmal nocturnal haemoglobinuria

Source: https://www.ema.europa.eu/en/medicines/human/orphan-designations/eu-3-20-2281